EMA orphan designation EU/3/20/2341
Active substance: autologous CD34+ cells transduced with a lentiviral vector encoding galactosidase alpha
Designated 2020-10-19. Orphan designation status: Withdrawn.
First published: 2021-02-16. Last updated: 2024-04-09.

Intended use: Treatment of Fabry disease

Source: https://www.ema.europa.eu/en/medicines/human/orphan-designations/eu-3-20-2341